EMA orphan designation EU/3/22/2634
Active substance: epcoritamab
Designated 2022-06-21. Orphan designation status: Withdrawn.
First published: 2022-09-22.

Intended use: Treatment of follicular lymphoma

Source: https://www.ema.europa.eu/en/medicines/human/orphan-designations/eu-3-22-2634